EMA orphan designation EU/3/12/965
Active substance: Ketoconazole
Designated 2012-04-23. Orphan designation status: Expired.
First published: 2012-05-03. Last updated: 2024-11-28.

Intended use: Treatment of Cushing's syndrome

Source: https://www.ema.europa.eu/en/medicines/human/orphan-designations/eu-3-12-965